EMA orphan designation EU/3/12/1091
Active substance: autologous CD34+ haematopoietic stem cells transduced with lentiviral vector encoding the human betaA-T87Q-globin gene (betibeglogene autotemcel)
Designated 2013-01-24. Orphan designation status: Withdrawn.
First published: 2013-03-01. Last updated: 2022-11-30.

Intended use: Treatment of beta thalassaemia intermedia and major

Source: https://www.ema.europa.eu/en/medicines/human/orphan-designations/eu-3-12-1091